EMA orphan designation EU/3/03/138
Active substance: alpha-1-acid glycoprotein
Designated 2003-03-20. Orphan designation status: Withdrawn.
First published: 2003-04-09. Last updated: 2010-06-23.

Intended use: Treatment of tricyclic anti-depressants poisoning

Source: https://www.ema.europa.eu/en/medicines/human/orphan-designations/eu-3-03-138